EMA orphan designation EU/3/24/3009
Active substance: 2'-O-4'-C-(S)-ethyl-P-thioadenylyl-(3'-O->5'-O)-2'-O-4'-C-(S)-ethyl-P-thioguanylyl-(3'-O->5'-O)-2'-O-methyl-P-thiocytidylyl-(3'-O->5'-O)-2'-fluoro-P-thioadenylyl-(3'-O->5'-O)-2'-fluoro-P-thiocytidylyl-(3'-O->5'-O)-2'-fluoro-P-thiouridylyl-(3'-O->5'-O)-2'-O-methyl-P-thiouridylyl-(3'-O->5'-O)-2'-O-4'-C-(S)-ethyl-P-thiouridylyl-(3'-O->5'-O)-2'-O-4'-C-(S)-ethyl-adenosine
Designated 2024-12-13. Orphan designation status: Positive.
First published: 2025-01-15.

Intended use: Treatment of autosomal dominant polycystic kidney disease

Source: https://www.ema.europa.eu/en/medicines/human/orphan-designations/eu-3-24-3009